EMA orphan designation EU/3/23/2776
Active substance: Fingolimod
Designated 2023-05-22. Orphan designation status: Positive.
First published: 2024-02-02.

Intended use: Treatment of hypomyelinating leukodystrophy-18

Source: https://www.ema.europa.eu/en/medicines/human/orphan-designations/eu-3-23-2776